EMA orphan designation EU/3/05/319
Active substance: Human Staphylococcus aureus immunoglobulin
Designated 2005-11-03. Orphan designation status: Positive.
First published: 2008-08-18. Last updated: 2008-08-18.

Intended use: Treatment of Staphylococcus aureus bacteraemia

Source: https://www.ema.europa.eu/en/medicines/human/orphan-designations/eu-3-05-319